EMA orphan designation EU/3/13/1143
Active substance: immortalised human C3A hepatoblastoma cells
Designated 2013-06-19. Orphan designation status: Withdrawn.
First published: 2013-07-11. Last updated: 2013-07-11.

Intended use: Treatment of acute liver failure

Source: https://www.ema.europa.eu/en/medicines/human/orphan-designations/eu-3-13-1143